EMA orphan designation EU/3/13/1107
Active substance: recombinant adeno-associated viral vector containing the human retinoschisin gene
Designated 2013-03-12. Orphan designation status: Positive.
First published: 2013-04-05. Last updated: 2022-10-06.

Intended use: Treatment of X-linked juvenile retinoschisis

Source: https://www.ema.europa.eu/en/medicines/human/orphan-designations/eu-3-13-1107